EMA orphan designation EU/3/20/2304
Active substance: Maralixibat chloride
Designated 2020-07-27. Orphan designation status: Positive.
First published: 2020-11-19. Last updated: 2021-09-24.

Intended use: Treatment of biliary atresia

Source: https://www.ema.europa.eu/en/medicines/human/orphan-designations/eu-3-20-2304